EMA orphan designation EU/3/12/1064
Active substance: alisertib
Designated 2012-11-08. Orphan designation status: Withdrawn.
First published: 2012-12-13. Last updated: 2017-02-07.

Intended use: Treatment of ovarian cancer

Source: https://www.ema.europa.eu/en/medicines/human/orphan-designations/eu-3-12-1064